EMA orphan designation EU/3/21/2423
Active substance: vatiquinone
Designated 2021-03-26. Orphan designation status: Positive.
First published: 2022-01-19.

Intended use: Treatment of Friedreich's ataxia

Source: https://www.ema.europa.eu/en/medicines/human/orphan-designations/eu-3-21-2423